EMA orphan designation EU/3/23/2875
Active substance: thiophene methylimidazole pentahydrogen
Designated 2024-01-12. Orphan designation status: Positive.
First published: 2024-04-11.

Intended use: Diagnosis of glioma

Source: https://www.ema.europa.eu/en/medicines/human/orphan-designations/eu-3-23-2875